EMA orphan designation EU/3/18/2122
Active substance: venglustat
Designated 2018-12-14. Orphan designation status: Withdrawn.
First published: 2019-03-08. Last updated: 2021-10-12.

Intended use: Treatment of autosomal dominant polycystic kidney disease

Source: https://www.ema.europa.eu/en/medicines/human/orphan-designations/eu-3-18-2122